EMA orphan designation EU/3/23/2826
Active substance: adeno-associated virus serotype 9 vector containing the human LAMP2 isoform B transgene
Designated 2023-08-16. Orphan designation status: Positive.
First published: 2024-01-30.

Intended use: Treatment of Danon disease

Source: https://www.ema.europa.eu/en/medicines/human/orphan-designations/eu-3-23-2826